EMA orphan designation EU/3/20/2333
Active substance: tipifarnib
Designated 2020-10-19. Orphan designation status: Withdrawn.
First published: 2021-02-12. Last updated: 2026-05-18.

Intended use: Treatment of peripheral T-cell lymphoma

Source: https://www.ema.europa.eu/en/medicines/human/orphan-designations/eu-3-20-2333